EMA orphan designation EU/3/01/037
Active substance: Humanised anti-HM1.24 monoclonal antibody
Designated 2001-05-10. Orphan designation status: Withdrawn.
First published: 2005-04-28. Last updated: 2005-04-28.

Intended use: Treatment of multiple myeloma

Source: https://www.ema.europa.eu/en/medicines/human/orphan-designations/eu-3-01-037